EMA orphan designation EU/3/14/1289
Active substance: recombinant human alpha-1-microglobulin
Designated 2014-07-04. Orphan designation status: Withdrawn.
First published: 2014-07-16. Last updated: 2021-05-11.

Intended use: Treatment of pre-eclampsia

Source: https://www.ema.europa.eu/en/medicines/human/orphan-designations/eu-3-14-1289